EMA orphan designation EU/3/22/2642: Nugalviq
Active substance: govorestat
Designated 2022-06-21. Orphan designation status: Positive. EMA product number: EMEA/H/C/006270.
First published: 2022-09-22. Last updated: 2023-05-10.

Intended use: Treatment of galactosaemia

Source: https://www.ema.europa.eu/en/medicines/human/orphan-designations/eu-3-22-2642